EMA orphan designation EU/3/20/2314
Active substance: Humanised IgG1 monoclonal antibody against human eotaxin-2; nebokitug
Designated 2020-08-21. Orphan designation status: Positive.
First published: 2020-11-25. Last updated: 2025-07-17.

Intended use: Treatment of primary sclerosing cholangitis

Source: https://www.ema.europa.eu/en/medicines/human/orphan-designations/eu-3-20-2314